EMA orphan designation EU/3/10/844
Active substance: axitinib
Designated 2011-02-23. Orphan designation status: Withdrawn.
First published: 2011-03-04. Last updated: 2017-02-10.

Intended use: Treatment of renal-cell carcinoma

Source: https://www.ema.europa.eu/en/medicines/human/orphan-designations/eu-3-10-844